EMA orphan designation EU/3/17/1873: Aspaveli
Active substance: Poly(oxy-1,2-ethanediyl),.alpha.-hydro-.omega.-hydroxy-,15,15'-diester with N-acetyl-L-isoleucyl-L-cysteinyl-L-valyl-1-methyl-L-tryptophyl-L-glutaminyl-L-.alpha.-aspartyl-L-tryptophylglycyl-L-alanyl-L-histidyl-L-arginyl-L-cysteinyl-L-threonyl-2-[2-(2-aminoethoxy)ethoxy]acetyl-N6-carboxy-L-lysinamide cyclic (2.fwdarw.12)-(disulfide); where two identical synthetic peptide domains are covalently linked at the ends of the polyethylene glycol chain
Designated 2017-05-22. Orphan designation status: Positive. EMA product number: EMEA/H/C/005553.
First published: 2017-06-29. Last updated: 2021-12-16.

Intended use: Treatment of paroxysmal nocturnal haemoglobinuria

Source: https://www.ema.europa.eu/en/medicines/human/orphan-designations/eu-3-17-1873